EMA orphan designation EU/3/13/1174
Active substance: trans-N1-((1R,2S)-2-phenylcyclopropyl)cyclohexane-1,4-diamine bis-hydrochloride
Designated 2013-08-05. Orphan designation status: Positive.
First published: 2013-09-13. Last updated: 2018-02-05.

Intended use: Treatment of acute myeloid leukaemia

Source: https://www.ema.europa.eu/en/medicines/human/orphan-designations/eu-3-13-1174